EMA orphan designation EU/3/22/2593
Active substance: adeno-associated virus serotype HSC15, containing human homology arms, expressing human phenylalanine hydroxylase
Designated 2022-03-16. Orphan designation status: Withdrawn.
First published: 2022-06-20. Last updated: 2024-04-09.

Intended use: Treatment of phenylalanine hydroxylase deficiency

Source: https://www.ema.europa.eu/en/medicines/human/orphan-designations/eu-3-22-2593